EMA orphan designation EU/3/10/835
Active substance: Maytansinoid-conjugated humanised monoclonal antibody against CD56
Designated 2011-02-23. Orphan designation status: Withdrawn.
First published: 2011-03-04. Last updated: 2015-03-06.

Intended use: Treatment of multiple myeloma

Source: https://www.ema.europa.eu/en/medicines/human/orphan-designations/eu-3-10-835